EMA orphan designation EU/3/17/1897
Active substance: tirapazamine
Designated 2017-07-17. Orphan designation status: Positive.
First published: 2017-08-14. Last updated: 2017-08-14.

Intended use: Treatment of hepatocellular carcinoma

Source: https://www.ema.europa.eu/en/medicines/human/orphan-designations/eu-3-17-1897